EMA orphan designation EU/3/18/1982
Active substance: N-(tert-butylcarbamoyl)-5-cyano-2-((4'-(difluoromethoxy)-[1,1'-biphenyl]-3-yl)oxy)benzenesulfonamide
Designated 2018-02-22. Orphan designation status: Positive.
First published: 2018-04-11. Last updated: 2020-04-08.

Intended use: Treatment of pulmonary arterial hypertension

Source: https://www.ema.europa.eu/en/medicines/human/orphan-designations/eu-3-18-1982